EMA orphan designation EU/3/13/1198
Active substance: recombinant human insulin receptor monoclonal antibody-fused iduronate 2-sulfatase
Designated 2013-11-13. Orphan designation status: Withdrawn.
First published: 2013-12-02. Last updated: 2022-02-18.

Intended use: Treatment of mucopolysaccharidosis type II (Hunter's syndrome)

Source: https://www.ema.europa.eu/en/medicines/human/orphan-designations/eu-3-13-1198